EMA orphan designation EU/3/12/1057
Active substance: naloxone hydrochloride dihydrate
Designated 2012-11-08. Orphan designation status: Positive.
First published: 2012-12-13. Last updated: 2012-12-13.

Intended use: Treatment of cutaneous T-cell lymphoma

Source: https://www.ema.europa.eu/en/medicines/human/orphan-designations/eu-3-12-1057